EMA orphan designation EU/3/22/2690
Active substance: Humanised IgG1 tetravalent monoclonal antibody against death receptor 5
Designated 2022-08-10. Orphan designation status: Positive.
First published: 2022-12-15.

Intended use: Treatment of chondrosarcoma

Source: https://www.ema.europa.eu/en/medicines/human/orphan-designations/eu-3-22-2690